EMA orphan designation EU/3/04/202
Active substance: HLA-A2 restricted CD8 T-cell line expressing MART-1 T-cell receptor
Designated 2004-06-21. Orphan designation status: Positive.
First published: 2009-06-15. Last updated: 2009-06-15.

Intended use: Treatment of MART-1 YES malignant melanoma in HLA-A2 YES patients

Source: https://www.ema.europa.eu/en/medicines/human/orphan-designations/eu-3-04-202